EMA orphan designation EU/3/19/2183: Xolremdi
Active substance: mavorixafor
Designated 2019-07-25. Orphan designation status: Positive. EMA product number: EMEA/H/C/006496.
First published: 2019-09-24. Last updated: 2024-04-08.

Intended use: Treatment of WHIM syndrome

Source: https://www.ema.europa.eu/en/medicines/human/orphan-designations/eu-3-19-2183